EMA orphan designation EU/3/18/2103
Designated 2018-12-14. Orphan designation status: Positive.
First published: 2019-03-12.

Intended use: Treatment of biliary atresia

Source: https://www.ema.europa.eu/en/medicines/human/orphan-designations/eu-3-18-2103